EMA orphan designation EU/3/08/571
Active substance: recombinant human minibody against complement component C5
Designated 2008-09-22. Orphan designation status: Withdrawn.
First published: 2009-04-02. Last updated: 2019-04-12.

Intended use: Treatment of atypical haemolytic uraemic syndrome (aHUS)

Source: https://www.ema.europa.eu/en/medicines/human/orphan-designations/eu-3-08-571